EMA orphan designation EU/3/20/2385: Rezzayo
Active substance: rezafungin acetate
Designated 2021-01-06. Orphan designation status: Positive. EMA product number: EMEA/H/C/005900.
First published: 2021-05-18. Last updated: 2022-06-30.

Intended use: Treatment of invasive candidiasis

Source: https://www.ema.europa.eu/en/medicines/human/orphan-designations/eu-3-20-2385